EMA orphan designation EU/3/11/883
Active substance: dextran sulfate
Designated 2011-08-05. Orphan designation status: Positive.
First published: 2011-08-16. Last updated: 2011-11-18.

Intended use: Treatment for mobilisation of progenitor cells prior to stem cell transplantation

Source: https://www.ema.europa.eu/en/medicines/human/orphan-designations/eu-3-11-883